EMA orphan designation EU/3/25/3087
Active substance: phosphorodiamidate morpholino oligonucleotide against the CUG repeat expansion of the DMPK gene mRNA transcript, conjugated to a cell penetrating peptide
Designated 2025-07-18. Orphan designation status: Positive.
First published: 2025-09-04.

Intended use: Treatment of dystrophic myotonia

Source: https://www.ema.europa.eu/en/medicines/human/orphan-designations/eu-3-25-3087